EMA orphan designation EU/3/04/231
Active substance: Deferoxamine mesilate
Designated 2004-10-20. Orphan designation status: Withdrawn.
First published: 2009-09-21. Last updated: 2013-10-08.

Intended use: Treatment of traumatic spinal cord injury

Source: https://www.ema.europa.eu/en/medicines/human/orphan-designations/eu-3-04-231